EMA orphan designation EU/3/24/2981
Active substance: sodium selenate
Designated 2024-11-10. Orphan designation status: Positive.
First published: 2024-11-20.

Intended use: Treatment of frontotemporal dementia

Source: https://www.ema.europa.eu/en/medicines/human/orphan-designations/eu-3-24-2981